EMA orphan designation EU/3/23/2824
Active substance: adeno-associated virus vector serotype 9 containing the PKP2 gene
Designated 2023-08-16. Orphan designation status: Positive.
First published: 2024-02-02.

Intended use: Treatment of arrhythmogenic right ventricular cardiomyopathy due to plakophilin-2 gene mutations

Source: https://www.ema.europa.eu/en/medicines/human/orphan-designations/eu-3-23-2824